EMA orphan designation EU/3/15/1590
Active substance: recombinant human monoclonal IgG1 antibody against programmed death ligand-1 (avelumab)
Designated 2015-12-14. Orphan designation status: Withdrawn.
First published: 2016-02-03. Last updated: 2019-10-15.

Intended use: Treatment of Merkel cell carcinoma

Source: https://www.ema.europa.eu/en/medicines/human/orphan-designations/eu-3-15-1590